EMA orphan designation EU/3/23/2767
Active substance: bezuclastinib
Designated 2023-03-20. Orphan designation status: Positive.
First published: 2024-02-01.

Intended use: Treatment of gastrointestinal stromal tumours

Source: https://www.ema.europa.eu/en/medicines/human/orphan-designations/eu-3-23-2767